EMA orphan designation EU/3/22/2603
Active substance: tiratricol
Designated 2022-04-13. Orphan designation status: Positive.
First published: 2022-07-19.

Intended use: Treatment of resistance to thyroid hormone type beta

Source: https://www.ema.europa.eu/en/medicines/human/orphan-designations/eu-3-22-2603